EMA orphan designation EU/3/26/3211
Active substance: hydroxocobalamin acetate
Designated 2026-03-25. Orphan designation status: Positive.
First published: 2026-04-22.

Intended use: Treatment of homocystinuria and/or methylmalonic acidaemia due to genetic defects of intracellular cobalamin processing

Source: https://www.ema.europa.eu/en/medicines/human/orphan-designations/eu-3-26-3211